EMA orphan designation EU/3/18/2023
Active substance: H-Arg-Pro-Lys-Pro-Gln-Gln-Phe-2Thi-Gly-Leu-Met(O2)-NH2-DOTA-225-Actinium
Designated 2018-05-25. Orphan designation status: Positive.
First published: 2018-07-24. Last updated: 2018-07-24.

Intended use: Treatment of glioma

Source: https://www.ema.europa.eu/en/medicines/human/orphan-designations/eu-3-18-2023